EMA orphan designation EU/3/10/839
Active substance: plitidepsin
Designated 2011-02-23. Orphan designation status: Withdrawn.
First published: 2011-03-07. Last updated: 2011-09-06.

Intended use: Treatment of post-polycythaemia vera myelofibrosis

Source: https://www.ema.europa.eu/en/medicines/human/orphan-designations/eu-3-10-839